EMA orphan designation EU/3/21/2446
Active substance: tislelizumab
Designated 2021-06-21. Orphan designation status: Withdrawn.
First published: 2022-05-11. Last updated: 2026-05-26.

Intended use: Treatment of nasopharyngeal cancer

Source: https://www.ema.europa.eu/en/medicines/human/orphan-designations/eu-3-21-2446